EMA orphan designation EU/3/21/2528
Active substance: D-lactic acid, glycolic acid
Designated 2021-11-12. Orphan designation status: Positive.
First published: 2022-06-07.

Intended use: Treatment of amyotrophic lateral sclerosis

Source: https://www.ema.europa.eu/en/medicines/human/orphan-designations/eu-3-21-2528